EMA orphan designation EU/3/08/552
Active substance: pegylated recombinant factor VIIa
Designated 2008-06-03. Orphan designation status: Withdrawn.
First published: 2008-08-18. Last updated: 2015-03-06.

Intended use: Treatment of haemophilia B

Source: https://www.ema.europa.eu/en/medicines/human/orphan-designations/eu-3-08-552